EMA orphan designation EU/3/12/1030
Active substance: vatreptacog alfa (activated)
Designated 2012-08-09. Orphan designation status: Withdrawn.
First published: 2012-09-27. Last updated: 2015-03-06.

Intended use: Treatment of haemophilia A

Source: https://www.ema.europa.eu/en/medicines/human/orphan-designations/eu-3-12-1030